EMA orphan designation EU/3/01/074
Active substance: Halofuginone hydrobromide
Designated 2001-12-11. Orphan designation status: Withdrawn.
First published: 2009-06-15. Last updated: 2019-11-20.

Intended use: Treatment of systemic sclerosis

Source: https://www.ema.europa.eu/en/medicines/human/orphan-designations/eu-3-01-074